EMA orphan designation EU/3/14/1309: Agamree
Active substance: 17α,21-Dihydroxy-16α-methyl-pregna-1,4,9(11)-triene-3,20-dione (vamorolone)
Designated 2014-08-22. Orphan designation status: Positive. EMA product number: EMEA/H/C/005679.
First published: 2014-10-01. Last updated: 2022-09-14.

Intended use: Treatment of Duchenne muscular dystrophy

Source: https://www.ema.europa.eu/en/medicines/human/orphan-designations/eu-3-14-1309